EMA orphan designation EU/3/01/034: Spanidin
Active substance: gusperimus trihydrochloride
Designated 2001-03-29. Orphan designation status: Positive. EMA product number: EMEA/H/C/000809.
First published: 2009-03-03. Last updated: 2011-11-18.

Intended use: Treatment of Wegener's granulomatosis

Source: https://www.ema.europa.eu/en/medicines/human/orphan-designations/eu-3-01-034